EMA orphan designation EU/3/09/675
Active substance: sequence-modified recombinant human factor VIIa
Designated 2009-10-09. Orphan designation status: Withdrawn.
First published: 2009-10-20. Last updated: 2016-01-19.

Intended use: Treatment of haemophilia A

Source: https://www.ema.europa.eu/en/medicines/human/orphan-designations/eu-3-09-675